EMA orphan designation EU/3/02/118
Active substance: recombinant glycoprotein gp350 of Epstein-Barr Virus
Designated 2002-10-22. Orphan designation status: Positive.
First published: 2002-12-17. Last updated: 2002-12-17.

Intended use: Prevention of post-transplantation lympho-proliferative disorders

Source: https://www.ema.europa.eu/en/medicines/human/orphan-designations/eu-3-02-118